EMA orphan designation EU/3/14/1367
Active substance: bazedoxifene acetate
Designated 2014-11-19. Orphan designation status: Positive.
First published: 2015-01-15. Last updated: 2015-01-15.

Intended use: Treatment of hereditary haemorrhagic telangiectasia

Source: https://www.ema.europa.eu/en/medicines/human/orphan-designations/eu-3-14-1367